EMA orphan designation EU/3/18/2043
Active substance: carboplatin; sodium valproate
Designated 2018-07-31. Orphan designation status: Positive.
First published: 2018-10-11. Last updated: 2022-03-18.

Intended use: Treatment of glioma

Source: https://www.ema.europa.eu/en/medicines/human/orphan-designations/eu-3-18-2043